EMA orphan designation EU/3/14/1348
Active substance: recombinant human bone morphogenetic protein 4
Designated 2014-10-15. Orphan designation status: Positive.
First published: 2014-11-12. Last updated: 2020-11-30.

Intended use: Treatment of glioma

Source: https://www.ema.europa.eu/en/medicines/human/orphan-designations/eu-3-14-1348